EMA orphan designation EU/3/07/508
Active substance: chimeric anti-interleukin-6 monoclonal antibody (siltuximab)
Designated 2007-11-30. Orphan designation status: Expired.
First published: 2008-07-29. Last updated: 2024-06-07.

Intended use: Treatment of Castleman's disease

Source: https://www.ema.europa.eu/en/medicines/human/orphan-designations/eu-3-07-508